EMA orphan designation EU/3/12/1019
Active substance: ramucirumab
Designated 2012-07-04. Orphan designation status: Withdrawn.
First published: 2012-07-24. Last updated: 2016-08-11.

Intended use: Treatment of hepatocellular carcinoma

Source: https://www.ema.europa.eu/en/medicines/human/orphan-designations/eu-3-12-1019